EMA orphan designation EU/3/22/2720
Active substance: (R)-deuteropioglitazone hydrochloride
Designated 2022-11-11. Orphan designation status: Positive.
First published: 2023-01-23.

Intended use: Treatment of adrenoleukodystrophy

Source: https://www.ema.europa.eu/en/medicines/human/orphan-designations/eu-3-22-2720